EMA orphan designation EU/3/17/1883
Active substance: pentamer formyl thiophene acetic acid
Designated 2017-06-20. Orphan designation status: Positive.
First published: 2017-07-17. Last updated: 2017-07-17.

Intended use: Treatment of Creutzfeldt-Jakob disease

Source: https://www.ema.europa.eu/en/medicines/human/orphan-designations/eu-3-17-1883